EMA orphan designation EU/3/25/3049
Active substance: humanised IgG1 monoclonal antibody against muscle specific kinase
Designated 2025-04-16. Orphan designation status: Positive.
First published: 2025-06-05.

Intended use: Treatment of spinal muscular atrophy

Source: https://www.ema.europa.eu/en/medicines/human/orphan-designations/eu-3-25-3049